EMA orphan designation EU/3/12/1039
Active substance: recombinant anti-CD3-bi-single-chain-Fv-diphtheria toxin fusion protein
Designated 2012-08-09. Orphan designation status: Positive.
First published: 2012-09-27. Last updated: 2022-02-04.

Intended use: Treatment of cutaneous T-cell lymphoma

Source: https://www.ema.europa.eu/en/medicines/human/orphan-designations/eu-3-12-1039